EMA orphan designation EU/3/25/3094
Active substance: resecabtagene autoleucel
Designated 2025-07-18. Orphan designation status: Positive.
First published: 2025-09-04.

Intended use: Treatment of idiopathic inflammatory myopathy

Source: https://www.ema.europa.eu/en/medicines/human/orphan-designations/eu-3-25-3094